EMA orphan designation EU/3/11/889
Active substance: pegylated recombinant Erwinia chrysanthemi L-asparaginase
Designated 2011-08-05. Orphan designation status: Positive.
First published: 2011-08-16. Last updated: 2012-05-24.

Intended use: Treatment of acute lymphoblastic leukaemia

Source: https://www.ema.europa.eu/en/medicines/human/orphan-designations/eu-3-11-889